EMA orphan designation EU/3/14/1378
Active substance: Diaspirin cross-linked haemoglobin
Designated 2014-11-19. Orphan designation status: Positive.
First published: 2015-01-16. Last updated: 2019-09-30.

Intended use: Treatment of hepatocellular carcinoma

Source: https://www.ema.europa.eu/en/medicines/human/orphan-designations/eu-3-14-1378